EMA orphan designation EU/3/18/2062
Active substance: bertilimumab
Designated 2018-11-24. Orphan designation status: Positive.
First published: 2018-12-20.

Intended use: Treatment of bullous pemphigoid

Source: https://www.ema.europa.eu/en/medicines/human/orphan-designations/eu-3-18-2062